EMA orphan designation EU/3/14/1282
Active substance: mixture of two adeno-associated viral vectors serotype 8 containing the 5'-half sequence of human MYO7A gene and the 3'-half sequence of human MYO7A gene
Designated 2014-07-04. Orphan designation status: Positive.
First published: 2014-07-16. Last updated: 2022-11-07.

Intended use: Treatment of Usher syndrome

Source: https://www.ema.europa.eu/en/medicines/human/orphan-designations/eu-3-14-1282